EMA orphan designation EU/3/21/2437
Active substance: synthetic 2′-O-(2-methoxyethyl)-modified antisense oligonucleotide linked to a triantennary cluster of N-acetyl galactosamine sugars targeting transmembrane protease, serine 6 mRNA
Designated 2021-05-20. Orphan designation status: Positive.
First published: 2022-03-18.

Intended use: Treatment of beta thalassaemia intermedia and major

Source: https://www.ema.europa.eu/en/medicines/human/orphan-designations/eu-3-21-2437